EMA orphan designation EU/3/23/2818
Active substance: allogeneic faecal microbiota, pooled
Designated 2023-08-16. Orphan designation status: Positive.
First published: 2024-02-01.

Intended use: Treatment in haematopoietic stem cell transplantation

Source: https://www.ema.europa.eu/en/medicines/human/orphan-designations/eu-3-23-2818